EMA orphan designation EU/3/20/2374: Spexotras
Active substance: trametinib dimethyl sulfoxide
Designated 2020-12-09. Orphan designation status: Positive. EMA product number: EMEA/H/C/005886.
First published: 2021-05-25.

Intended use: Treatment of glioma

Source: https://www.ema.europa.eu/en/medicines/human/orphan-designations/eu-3-20-2374